EMA orphan designation EU/3/10/775
Active substance: autologous bone-marrow-derived mononuclear-cell fraction
Designated 2010-09-20. Orphan designation status: Positive.
First published: 2010-10-18. Last updated: 2013-10-08.

Intended use: Treatment of thromboangiitis obliterans (Buerger's disease)

Source: https://www.ema.europa.eu/en/medicines/human/orphan-designations/eu-3-10-775